EMA orphan designation EU/3/12/1007
Active substance: 17-(Dimethylaminoethylamino)-17-demethoxygeldanamycin
Designated 2012-11-28. Orphan designation status: Withdrawn.
First published: 2012-12-17. Last updated: 2016-08-11.

Intended use: Treatment of retinitis pigmentosa

Source: https://www.ema.europa.eu/en/medicines/human/orphan-designations/eu-3-12-1007